EMA orphan designation EU/3/11/856
Active substance: recombinant thymidine phosphorylase encapsulated in autologous erythrocytes
Designated 2011-04-15. Orphan designation status: Positive.
First published: 2011-04-26. Last updated: 2011-04-26.

Intended use: Treatment of mitochondrial neurogastrointestinal encephalomyopathy (MNGIE)

Source: https://www.ema.europa.eu/en/medicines/human/orphan-designations/eu-3-11-856